EMA orphan designation EU/3/09/630
Active substance: skin equivalent graft genetically corrected with a COL7A1-encoding SIN retroviral vector
Designated 2009-04-30. Orphan designation status: Positive.
First published: 2009-05-12. Last updated: 2014-10-07.

Intended use: Treatment of dystrophic epidermolysis bullosa

Source: https://www.ema.europa.eu/en/medicines/human/orphan-designations/eu-3-09-630